EMA orphan designation EU/3/16/1759
Active substance: recombinant adeno-associated viral vector encoding a human micro-dystrophin gene under the control of a muscle specific promoter
Designated 2016-10-14. Orphan designation status: Positive.
First published: 2016-11-15. Last updated: 2024-04-08.

Intended use: Treatment of Duchenne muscular dystrophy

Source: https://www.ema.europa.eu/en/medicines/human/orphan-designations/eu-3-16-1759